EMA orphan designation EU/3/15/1446
Active substance: N-(3-(4-(3-(diisobutylamino)propyl)piperazin-1-yl)propyl)-1H-benzo[d]imidazol-2-amine disulfate salt
Designated 2015-02-12. Orphan designation status: Positive.
First published: 2015-04-09. Last updated: 2019-07-10.

Intended use: Treatment of progressive supranuclear palsy

Source: https://www.ema.europa.eu/en/medicines/human/orphan-designations/eu-3-15-1446